EMA orphan designation EU/3/19/2168
Active substance: (S)-6-hydroxy-2,5,7,8-tetramethyl-N-((R)-piperidine-3-yl)chroman-2-carboxamide hydrochloride
Designated 2019-06-28. Orphan designation status: Withdrawn.
First published: 2019-10-02. Last updated: 2023-03-27.

Intended use: Treatment of maternally-inherited diabetes and deafness

Source: https://www.ema.europa.eu/en/medicines/human/orphan-designations/eu-3-19-2168